EMA orphan designation EU/3/08/562
Active substance: topotecan hydrochloride
Designated 2008-09-05. Orphan designation status: Positive.
First published: 2009-04-24. Last updated: 2009-04-24.

Intended use: Treatment of glioma

Source: https://www.ema.europa.eu/en/medicines/human/orphan-designations/eu-3-08-562